EMA orphan designation EU/3/15/1481
Active substance: phenol, 4-[2-(aminomethyl)-4-thiazolyl]-2,6-bis(1,1-dimethylethyl) monohydrochloride
Designated 2015-04-24. Orphan designation status: Withdrawn.
First published: 2015-05-22. Last updated: 2017-10-19.

Intended use: Treatment of Huntington’s disease

Source: https://www.ema.europa.eu/en/medicines/human/orphan-designations/eu-3-15-1481